EMA orphan designation EU/3/23/2838
Active substance: Humanised IgG1 monoclonal antibody against TfR1 conjugated to exon 44 specific phosphorodiamidate morpholino oligonucleotide via a non-cleavable linker
Designated 2023-10-13. Orphan designation status: Positive.
First published: 2024-02-01.

Intended use: Treatment of Duchenne muscular dystrophy

Source: https://www.ema.europa.eu/en/medicines/human/orphan-designations/eu-3-23-2838